EMA orphan designation EU/3/26/3205
Active substance: povetacicept
Designated 2026-02-23. Orphan designation status: Positive.
First published: 2026-04-13.

Intended use: Treatment of primary membranous nephropathy

Source: https://www.ema.europa.eu/en/medicines/human/orphan-designations/eu-3-26-3205-0